EMA orphan designation EU/3/07/510
Active substance: artesunate
Designated 2007-12-07. Orphan designation status: Positive.
First published: 2008-07-02. Last updated: 2013-10-17.

Intended use: Treatment of malaria

Source: https://www.ema.europa.eu/en/medicines/human/orphan-designations/eu-3-07-510